EMA orphan designation EU/3/21/2480
Active substance: Bardoxolone methyl
Designated 2021-08-20. Orphan designation status: Positive.
First published: 2022-02-25. Last updated: 2023-01-30.

Intended use: Treatment of autosomal dominant polycystic kidney disease

Source: https://www.ema.europa.eu/en/medicines/human/orphan-designations/eu-3-21-2480